EMA orphan designation EU/3/07/490
Active substance: human autologous bone-forming cells derived from bone marrow stem cells
Designated 2007-10-29. Orphan designation status: Withdrawn.
First published: 2008-01-17. Last updated: 2019-11-20.

Intended use: Treatment of non-traumatic osteonecrosis

Source: https://www.ema.europa.eu/en/medicines/human/orphan-designations/eu-3-07-490